EMA orphan designation EU/3/19/2215
Active substance: paclitaxel
Designated 2019-10-17. Orphan designation status: Positive.
First published: 2020-01-23. Last updated: 2021-09-16.

Intended use: Treatment of soft tissue sarcoma

Source: https://www.ema.europa.eu/en/medicines/human/orphan-designations/eu-3-19-2215